EMA orphan designation EU/3/10/752
Active substance: velaglucerase alfa
Designated 2010-06-06. Orphan designation status: Expired.
First published: 2010-06-23. Last updated: 2022-09-09.

Intended use: Treatment of Gaucher disease

Source: https://www.ema.europa.eu/en/medicines/human/orphan-designations/eu-3-10-752